EMA orphan designation EU/3/16/1799
Active substance: cabiralizumab
Designated 2016-12-12. Orphan designation status: Withdrawn.
First published: 2017-01-09. Last updated: 2020-08-12.

Intended use: Treatment of tenosynovial giant cell tumour, localised and diffuse type

Source: https://www.ema.europa.eu/en/medicines/human/orphan-designations/eu-3-16-1799